EMA orphan designation EU/3/17/1859
Active substance: Phosphoinositide 3-kinase gamma peptide
Designated 2017-03-20. Orphan designation status: Positive.
First published: 2017-05-05. Last updated: 2017-05-05.

Intended use: Treatment of cystic fibrosis

Source: https://www.ema.europa.eu/en/medicines/human/orphan-designations/eu-3-17-1859